EMA orphan designation EU/3/09/683
Active substance: cholic acid
Designated 2009-10-29. Orphan designation status: Withdrawn.
First published: 2009-11-18. Last updated: 2020-07-29.

Intended use: Treatment of inborn errors in primary bile acid synthesis responsive to treatment with cholic acid

Source: https://www.ema.europa.eu/en/medicines/human/orphan-designations/eu-3-09-683